EMA orphan designation EU/3/22/2591
Active substance: emactuzumab
Designated 2022-03-16. Orphan designation status: Positive.
First published: 2022-06-20.

Intended use: Treatment of tenosynovial giant cell tumour, localised and diffuse type

Source: https://www.ema.europa.eu/en/medicines/human/orphan-designations/eu-3-22-2591